EMA orphan designation EU/3/14/1315
Active substance: recombinant human apolipoprotein A-I in a complex with phospholipids
Designated 2014-08-22. Orphan designation status: Positive.
First published: 2014-10-01. Last updated: 2014-10-01.

Intended use: Treatment of apolipoprotein A-I deficiency

Source: https://www.ema.europa.eu/en/medicines/human/orphan-designations/eu-3-14-1315